EMA orphan designation EU/3/23/2850
Active substance: setanaxib
Designated 2023-11-08. Orphan designation status: Positive.
First published: 2024-02-01.

Intended use: Treatment of Alport syndrome

Source: https://www.ema.europa.eu/en/medicines/human/orphan-designations/eu-3-23-2850